EMA orphan designation EU/3/15/1528
Active substance: synthetic double-stranded RNA oligonucleotide specific to hydroxyacid oxidase 1 gene
Designated 2015-07-28. Orphan designation status: Withdrawn.
First published: 2015-08-18. Last updated: 2018-06-13.

Intended use: Treatment of primary hyperoxaluria type 1

Source: https://www.ema.europa.eu/en/medicines/human/orphan-designations/eu-3-15-1528